EMA orphan designation EU/3/14/1244
Active substance: ruxolitinib
Designated 2014-02-19. Orphan designation status: Withdrawn.
First published: 2014-04-02. Last updated: 2015-05-08.

Intended use: Treatment of polycythaemia vera

Source: https://www.ema.europa.eu/en/medicines/human/orphan-designations/eu-3-14-1244